EMA orphan designation EU/3/04/227
Active substance: 1, 1'-[1,4-Phenylenebis (methylene)]-bis-1,4,8,11-tetraazacyclotetradecane (plerixafor)
Designated 2004-10-20. Orphan designation status: Expired.
First published: 2008-08-18. Last updated: 2021-08-10.

Intended use: Treatment to mobilize progenitor cells prior to stem cell transplantation

Source: https://www.ema.europa.eu/en/medicines/human/orphan-designations/eu-3-04-227